EMA orphan designation EU/3/21/2544
Active substance: ribonucleoprotein complex composed of two sgRNA and a Cas9 nuclease targeting the human COL7A1 gene
Designated 2021-12-10. Orphan designation status: Positive.
First published: 2022-04-20.

Intended use: Treatment of epidermolysis bullosa

Source: https://www.ema.europa.eu/en/medicines/human/orphan-designations/eu-3-21-2544